EMA orphan designation EU/3/15/1458: Elahere
Active substance: Humanised anti-folate receptor 1 monoclonal antibody conjugated to maytansinoid DM4
Designated 2015-03-19. Orphan designation status: Positive. EMA product number: EMEA/H/C/005036.
First published: 2015-05-11. Last updated: 2024-10-28.

Intended use: Treatment of ovarian cancer

Source: https://www.ema.europa.eu/en/medicines/human/orphan-designations/eu-3-15-1458